EMA orphan designation EU/3/19/2197
Active substance: recombinant self-complementary adeno-associated viral vector serotype 9 containing the human CLN6 gene
Designated 2019-08-21. Orphan designation status: Positive.
First published: 2020-01-16. Last updated: 2024-10-22.

Intended use: Treatment of neuronal ceroid lipofuscinosis

Source: https://www.ema.europa.eu/en/medicines/human/orphan-designations/eu-3-19-2197